EMA orphan designation EU/3/16/1787: Prohippur
Active substance: sodium benzoate
Designated 2016-11-18. Orphan designation status: Positive. EMA product number: EMEA/H/C/004150.
First published: 2016-12-14. Last updated: 2016-12-14.

Intended use: Treatment of argininosuccinic aciduria

Source: https://www.ema.europa.eu/en/medicines/human/orphan-designations/eu-3-16-1787